EMA orphan designation EU/3/21/2564
Active substance: 5-((4'-(3,3-difluorocyclobutyl)-[1,1'-biphenyl]-4-yl)oxy)-1H-1,2,3-triazole-4-carboxylic acid
Designated 2022-01-14. Orphan designation status: Withdrawn.
First published: 2022-06-09.

Intended use: Treatment of primary hyperoxaluria

Source: https://www.ema.europa.eu/en/medicines/human/orphan-designations/eu-3-21-2564